EMA orphan designation EU/3/18/2120
Active substance: pevonedistat
Designated 2018-12-14. Orphan designation status: Withdrawn.
First published: 2019-03-12. Last updated: 2022-02-18.

Intended use: Treatment of myelodysplastic syndromes

Source: https://www.ema.europa.eu/en/medicines/human/orphan-designations/eu-3-18-2120